EMA orphan designation EU/3/17/1952
Active substance: modified messenger ribonucleic acid encoding human argininosuccinate lyase enzyme encapsulated into lipid nanoparticles
Designated 2017-12-12. Orphan designation status: Positive.
First published: 2018-01-23. Last updated: 2018-01-23.

Intended use: Treatment of argininosuccinic aciduria

Source: https://www.ema.europa.eu/en/medicines/human/orphan-designations/eu-3-17-1952